EMA orphan designation EU/3/21/2464: Kymriah
Active substance: tisagenlecleucel
Designated 2021-07-19. Orphan designation status: Positive. EMA product number: EMEA/H/C/004090.
First published: 2021-11-12. Last updated: 2022-05-19.

Intended use: Treatment of follicular lymphoma

Source: https://www.ema.europa.eu/en/medicines/human/orphan-designations/eu-3-21-2464